EMA orphan designation EU/3/16/1629
Active substance: exenatide
Designated 2016-03-21. Orphan designation status: Positive.
First published: 2016-05-02. Last updated: 2021-09-16.

Intended use: Treatment of idiopathic intracranial hypertension

Source: https://www.ema.europa.eu/en/medicines/human/orphan-designations/eu-3-16-1629